EMA orphan designation EU/3/15/1503
Active substance: allogeneic ex-vivo-expanded human umbilical cord blood-derived mesenchymal stem cells
Designated 2015-06-19. Orphan designation status: Positive.
First published: 2015-07-27. Last updated: 2015-07-27.

Intended use: Prevention of bronchopulmonary dysplasia

Source: https://www.ema.europa.eu/en/medicines/human/orphan-designations/eu-3-15-1503